EMA orphan designation EU/3/14/1338
Active substance: adeno-associated viral vector serotype 8 containing the human UGT1A1 gene (volrubigene ralaparvovec)
Designated 2014-10-15. Orphan designation status: Positive.
First published: 2014-11-12. Last updated: 2014-11-12.

Intended use: Treatment of Crigler-Najjar syndrome

Source: https://www.ema.europa.eu/en/medicines/human/orphan-designations/eu-3-14-1338